EMA orphan designation EU/3/21/2440
Active substance: autologous mobilised peripheral blood-derived CD34+ cells transduced ex vivo with a self-inactivating lentiviral vector containing a normal version of the coding region of the IL2RG gene
Designated 2021-05-20. Orphan designation status: Withdrawn.
First published: 2022-03-18. Last updated: 2024-11-19.

Intended use: Treatment of X-linked severe combined immunodeficiency

Source: https://www.ema.europa.eu/en/medicines/human/orphan-designations/eu-3-21-2440